EMA orphan designation EU/3/20/2353
Active substance: zanidatamab
Designated 2020-11-13. Orphan designation status: Positive.
First published: 2021-02-15. Last updated: 2023-06-27.

Intended use: Treatment of gastric cancer

Source: https://www.ema.europa.eu/en/medicines/human/orphan-designations/eu-3-20-2353